EMA orphan designation EU/3/00/014
Active substance: iloprost
Designated 2000-12-30. Orphan designation status: Expired.
First published: 2009-03-12. Last updated: 2013-10-08.

Intended use: Treatment of primary and of the following forms of secondary pulmonary hypertension…

Source: https://www.ema.europa.eu/en/medicines/human/orphan-designations/eu-3-00-014